EMA orphan designation EU/3/22/2677
Active substance: H-Lys-Lys-Gly-Asp-Asn-Ile-Met-Val-Thr-Phe-Arg-Asn-Gln-Ala-Ser-Arg-Pro-Tyr-Gly-Lys-Lys-OH
Designated 2022-08-10. Orphan designation status: Withdrawn.
First published: 2022-12-15. Last updated: 2024-11-19.

Intended use: Treatment of haemophilia A

Source: https://www.ema.europa.eu/en/medicines/human/orphan-designations/eu-3-22-2677